EMA orphan designation EU/3/14/1276: Cresemba
Active substance: Isavuconazonium sulfate
Designated 2014-06-04. Orphan designation status: Positive. EMA product number: EMEA/H/C/002734.
First published: 2014-07-10. Last updated: 2019-05-21.

Intended use: Treatment of mucormycosis

Source: https://www.ema.europa.eu/en/medicines/human/orphan-designations/eu-3-14-1276